EMA orphan designation EU/3/12/1021
Active substance: 1-[(2-Chloro-4-methoxyphenoxy)methyl]-4-[(2,6-dichlorophenoxy)methyl]benzene
Designated 2012-07-17. Orphan designation status: Positive.
First published: 2012-08-31. Last updated: 2013-03-12.

Intended use: Prevention of poliomyelitis in patients with immunodeficiencies deemed at risk

Source: https://www.ema.europa.eu/en/medicines/human/orphan-designations/eu-3-12-1021